EMA orphan designation EU/3/10/782
Active substance: nafamostat mesilate
Designated 2010-09-20. Orphan designation status: Positive.
First published: 2010-10-18. Last updated: 2010-10-18.

Intended use: Treatment of cystic fibrosis

Source: https://www.ema.europa.eu/en/medicines/human/orphan-designations/eu-3-10-782